EMA orphan designation EU/3/06/376
Active substance: recombinant P-selectin glycoprotein immunoglobulin
Designated 2006-05-22. Orphan designation status: Positive.
First published: 2009-02-24. Last updated: 2011-06-01.

Intended use: Prevention of post transplantation graft dysfunction

Source: https://www.ema.europa.eu/en/medicines/human/orphan-designations/eu-3-06-376